EMA orphan designation EU/3/04/252
Active substance: Val-Leu-Gln-Glu-Leu-Asn-Val-Thr-Val (Pr1 nanopeptide, sequence 169-177, of proteinase 3)
Designated 2004-12-21. Orphan designation status: Withdrawn.
First published: 2006-01-06. Last updated: 2009-11-19.

Intended use: Treatment of chronic myeloid leukaemia

Source: https://www.ema.europa.eu/en/medicines/human/orphan-designations/eu-3-04-252